EMA orphan designation EU/3/25/3169
Active substance: N-[[(2S)-4-[(4-Methyl-1H-imidazol-5-yl)methyl]-3-oxo-2-(phenylmethyl)-1-piperazinyl]carbonyl]-L-leucine trihydrate
Designated 2025-12-09. Orphan designation status: Positive.
First published: 2026-03-27.

Intended use: Treatment of cutaneous T-cell lymphoma

Source: https://www.ema.europa.eu/en/medicines/human/orphan-designations/eu-3-25-3169